EMA orphan designation EU/3/11/860: Lumevoq
Active substance: adeno-associated viral vector containing the human NADH-dehydrogenase-4 gene
Designated 2011-05-13. Orphan designation status: Positive. EMA product number: EMEA/H/C/005047.
First published: 2011-05-27. Last updated: 2016-04-11.

Intended use: Treatment of Leber's hereditary optic neuropathy

Source: https://www.ema.europa.eu/en/medicines/human/orphan-designations/eu-3-11-860